EMA orphan designation EU/3/25/3067
Active substance: sodium 2-(3'(-3-(1-(4-(tert-butyl)benzyl)-4-ethyl-5-oxo-4,5-dihydro-1H-1,2,4-triazol-3-yl)propyl)-4-ethoxy-[1,1'-biphenyl]-3-yl)acetate
Designated 2025-06-20. Orphan designation status: Positive.
First published: 2025-07-17.

Intended use: Treatment of hepatocellular carcinoma

Source: https://www.ema.europa.eu/en/medicines/human/orphan-designations/eu-3-25-3067